EMA orphan designation EU/3/20/2357
Active substance: tislelizumab
Designated 2020-11-13. Orphan designation status: Withdrawn.
First published: 2021-02-15. Last updated: 2021-12-14.

Intended use: Treatment of oesophageal cancer

Source: https://www.ema.europa.eu/en/medicines/human/orphan-designations/eu-3-20-2357